EMA orphan designation EU/3/23/2771
Active substance: adeno-associated viral vector serotype 1 containing the 3' portion of human OTOF gene, Adeno-associated viral vector serotype 1 containing the 5' portion of human OTOF gene
Designated 2023-03-20. Orphan designation status: Positive.
First published: 2024-02-01. Last updated: 2024-10-31.

Intended use: Treatment of otoferlin gene-mediated hearing loss

Source: https://www.ema.europa.eu/en/medicines/human/orphan-designations/eu-3-23-2771